EMA orphan designation EU/3/03/169
Active substance: human immunoglobulin
Designated 2003-10-20. Orphan designation status: Withdrawn.
First published: 2004-04-26. Last updated: 2013-10-08.

Intended use: Treatment of dermatomyositis

Source: https://www.ema.europa.eu/en/medicines/human/orphan-designations/eu-3-03-169